EMA orphan designation EU/3/14/1345: Isturisa
Active substance: osilodrostat
Designated 2014-10-15. Orphan designation status: Positive. EMA product number: EMEA/H/C/004821.
First published: 2014-11-12. Last updated: 2020-07-07.

Intended use: Treatment of Cushing's syndrome

Source: https://www.ema.europa.eu/en/medicines/human/orphan-designations/eu-3-14-1345